EMA orphan designation EU/3/00/007
Active substance: N-carbamyl-L-glutamic acid
Designated 2000-10-18. Orphan designation status: Expired.
First published: 2009-03-03. Last updated: 2014-07-25.

Intended use: Treatment of N-acetylglutamate synthetase (NAGS) deficiency

Source: https://www.ema.europa.eu/en/medicines/human/orphan-designations/eu-3-00-007